EMA orphan designation EU/3/18/2050: Koselugo
Active substance: selumetinib
Designated 2018-07-31. Orphan designation status: Positive. EMA product number: EMEA/H/C/005244.
First published: 2018-10-11. Last updated: 2021-09-15.

Intended use: Treatment of neurofibromatosis type 1

Source: https://www.ema.europa.eu/en/medicines/human/orphan-designations/eu-3-18-2050